EMA orphan designation EU/3/26/3216
Active substance: exaluren sulfate
Designated 2026-03-25. Orphan designation status: Positive.
First published: 2026-04-22.

Intended use: Treatment of Alport syndrome

Source: https://www.ema.europa.eu/en/medicines/human/orphan-designations/eu-3-26-3216